EMA orphan designation EU/3/07/482
Active substance: sulfonated monophosphorylated mannose oligosaccharide
Designated 2007-09-14. Orphan designation status: Withdrawn.
First published: 2009-10-15. Last updated: 2009-10-15.

Intended use: Treatment of hepatocellular carcinoma

Source: https://www.ema.europa.eu/en/medicines/human/orphan-designations/eu-3-07-482